EMA orphan designation EU/3/03/172
Active substance: trientine dihydrochloride
Designated 2003-10-24. Orphan designation status: Withdrawn.
First published: 2004-04-26. Last updated: 2019-07-29.

Intended use: Treatment of Wilson's disease

Source: https://www.ema.europa.eu/en/medicines/human/orphan-designations/eu-3-03-172